EMA orphan designation EU/3/24/2914
Active substance: Humanised IgG1 (K322A) monoclonal antibody against disialoganglioside GD2
Designated 2024-04-16. Orphan designation status: Positive.
First published: 2024-07-26.

Intended use: Treatment of neuroblastoma

Source: https://www.ema.europa.eu/en/medicines/human/orphan-designations/eu-3-24-2914